EMA orphan designation EU/3/12/1089
Active substance: choline tetrathiomolybdate
Designated 2013-01-24. Orphan designation status: Positive.
First published: 2013-03-01. Last updated: 2019-11-20.

Intended use: Treatment of Wilson's disease

Source: https://www.ema.europa.eu/en/medicines/human/orphan-designations/eu-3-12-1089